EMA orphan designation EU/3/19/2242: Casgevy
Active substance: autologous CD34+ hematopoietic stem cells with a CRISPR-edited erythroid enhancer region of the BCL11A gene
Designated 2020-01-09. Orphan designation status: Positive. EMA product number: EMEA/H/C/005763.
First published: 2020-05-19. Last updated: 2022-01-25.

Intended use: Treatment of sickle cell disease

Source: https://www.ema.europa.eu/en/medicines/human/orphan-designations/eu-3-19-2242